EMA orphan designation EU/3/19/2202
Active substance: (16E)-14-methyl-20-oxa-5,7,14,26-tetraaza-tetracyclo[19.3.1.1(2,6).1(8,12)]heptacosa-1(25),2(26),3,5,8(27),9,11,16,21,23-decaene–citric acid
Designated 2019-10-17. Orphan designation status: Positive.
First published: 2020-01-21. Last updated: 2020-08-27.

Intended use: Treatment of glioma

Source: https://www.ema.europa.eu/en/medicines/human/orphan-designations/eu-3-19-2202